EMA orphan designation EU/3/21/2433
Active substance: adeno-associated viral vector serotype 2.5T encoding the human cystic fibrosis transmembrane conductance regulator with a partial deletion in the R domain
Designated 2021-05-20. Orphan designation status: Positive.
First published: 2022-03-18.

Intended use: Treatment of cystic fibrosis

Source: https://www.ema.europa.eu/en/medicines/human/orphan-designations/eu-3-21-2433